EMA orphan designation EU/3/18/1998
Active substance: patidegib
Orphan designation status: Positive.
First published: 2017-05-08. Last updated: 2023-02-24.

Intended use: Treatment of naevoid basal-cell carcinoma syndrome (Gorlin syndrome)

Source: https://www.ema.europa.eu/en/medicines/human/orphan-designations/eu-3-18-1998